EMA orphan designation EU/3/18/2014
Active substance: 1-(3-{4-[3,4-difluoro-2-(trifluoromethyl)phenyl]piperidine-1-carbonyl}-1H,4H,5H,6H,7H-pyrazolo[3,4- c]pyridin-6-yl)ethan-1-one (Tinlarebant)
Designated 2018-05-25. Orphan designation status: Positive.
First published: 2018-07-23. Last updated: 2022-12-01.

Intended use: Treatment of Stargardt's disease

Source: https://www.ema.europa.eu/en/medicines/human/orphan-designations/eu-3-18-2014